EMA orphan designation EU/3/20/2387
Active substance: setanaxib
Designated 2021-01-06. Orphan designation status: Positive.
First published: 2021-05-18. Last updated: 2022-08-30.

Intended use: Treatment of primary biliary cholangitis

Source: https://www.ema.europa.eu/en/medicines/human/orphan-designations/eu-3-20-2387